EMA orphan designation EU/3/04/221
Active substance: 5,10-Methylene-tetrahydrofolic acid
Designated 2004-09-02. Orphan designation status: Withdrawn.
First published: 2009-10-15. Last updated: 2009-10-15.

Intended use: Treatment of pancreatic cancer in combination with 5-fluorouracil

Source: https://www.ema.europa.eu/en/medicines/human/orphan-designations/eu-3-04-221